EMA orphan designation EU/3/10/816
Active substance: 7-beta-hydroxy cholesteryl-3-beta-oleate
Designated 2010-12-17. Orphan designation status: Positive.
First published: 2011-01-19. Last updated: 2023-09-22.

Intended use: Treatment of glioma

Source: https://www.ema.europa.eu/en/medicines/human/orphan-designations/eu-3-10-816